EMA orphan designation EU/3/03/163
Active substance: 5,6,7,8-Tetrahydrobiopterin
Designated 2003-10-02. Orphan designation status: Positive.
First published: 2007-02-23. Last updated: 2007-02-23.

Intended use: Treatment of hyperphenylalaninaemia

Source: https://www.ema.europa.eu/en/medicines/human/orphan-designations/eu-3-03-163